EMA orphan designation EU/3/06/427
Active substance: Fenretinide
Designated 2007-01-30. Orphan designation status: Withdrawn.
First published: 2008-07-29. Last updated: 2011-03-25.

Intended use: Treatment of soft tissue sarcoma

Source: https://www.ema.europa.eu/en/medicines/human/orphan-designations/eu-3-06-427